EMA orphan designation EU/3/23/2863
Active substance: brogidirsen
Designated 2023-12-13. Orphan designation status: Positive.
First published: 2024-02-02.

Intended use: Treatment of Duchenne muscular dystrophy

Source: https://www.ema.europa.eu/en/medicines/human/orphan-designations/eu-3-23-2863